EMA orphan designation EU/3/13/1156
Active substance: recombinant human monoclonal antibody against hepatitis-B virus (active ingredient lenvervimab)
Designated 2013-08-15. Orphan designation status: Positive.
First published: 2013-08-22. Last updated: 2020-02-04.

Intended use: Prevention of hepatitis-B re-infection following liver transplantation

Source: https://www.ema.europa.eu/en/medicines/human/orphan-designations/eu-3-13-1156